EMA orphan designation EU/3/22/2615
Active substance: Ibudilast
Designated 2022-05-16. Orphan designation status: Positive.
First published: 2022-12-14.

Intended use: Treatment of fragile X syndrome

Source: https://www.ema.europa.eu/en/medicines/human/orphan-designations/eu-3-22-2615